EMA orphan designation EU/3/26/3218
Active substance: lisaftoclax
Designated 2026-04-13. Orphan designation status: Positive.
First published: 2026-04-22.

Intended use: Treatment of myelodysplastic syndromes

Source: https://www.ema.europa.eu/en/medicines/human/orphan-designations/eu-3-26-3218